EMA orphan designation EU/3/06/353
Active substance: 26 base single stranded phosphodiester DNA oligonucleotide
Designated 2006-02-16. Orphan designation status: Withdrawn.
First published: 2009-09-17. Last updated: 2011-05-18.

Intended use: Treatment of renal-cell carcinoma

Source: https://www.ema.europa.eu/en/medicines/human/orphan-designations/eu-3-06-353